EMA orphan designation EU/3/10/814
Active substance: recombinant human von Willebrand factor
Designated 2010-11-26. Orphan designation status: Withdrawn.
First published: 2010-12-17. Last updated: 2018-09-21.

Intended use: Treatment of von Willebrand disease

Source: https://www.ema.europa.eu/en/medicines/human/orphan-designations/eu-3-10-814